EMA orphan designation EU/3/04/214: Rydapt
Active substance: Midostaurin
Designated 2004-07-29. Orphan designation status: Positive. EMA product number: EMEA/H/C/004095.
First published: 2005-10-11. Last updated: 2018-05-29.

Intended use: Treatment of acute myeloid leukaemia

Source: https://www.ema.europa.eu/en/medicines/human/orphan-designations/eu-3-04-214